EMA orphan designation EU/3/21/2419
Active substance: single guide RNA targeting the human TTR gene, Ziclumeran
Designated 2021-03-26. Orphan designation status: Positive.
First published: 2022-01-19. Last updated: 2025-06-05.

Intended use: Treatment of ATTR amyloidosis

Source: https://www.ema.europa.eu/en/medicines/human/orphan-designations/eu-3-21-2419